EMA orphan designation EU/3/04/205
Active substance: suberolylanilide hydroxamic acid
Designated 2004-06-21. Orphan designation status: Withdrawn.
First published: 2005-10-11. Last updated: 2013-03-12.

Intended use: Treatment of cutaneous T-cell lymphoma

Source: https://www.ema.europa.eu/en/medicines/human/orphan-designations/eu-3-04-205